EMA orphan designation EU/3/09/643
Active substance: Desipramine chlorhydrate
Designated 2009-06-12. Orphan designation status: Withdrawn.
First published: 2009-07-01. Last updated: 2017-08-16.

Intended use: Treatment of Rett syndrome

Source: https://www.ema.europa.eu/en/medicines/human/orphan-designations/eu-3-09-643